EMA orphan designation EU/3/22/2606
Active substance: adeno-associated viral vector serotype 8 encoding B-domain deleted liver specific codon optimized bioengineered chimeric human porcine factor VIII, under a synthetic hepatic combinatorial bundle promoter
Designated 2022-04-13. Orphan designation status: Positive.
First published: 2022-07-19. Last updated: 2024-04-08.

Intended use: Treatment of haemophilia A

Source: https://www.ema.europa.eu/en/medicines/human/orphan-designations/eu-3-22-2606